EMA orphan designation EU/3/04/212
Active substance: defibrotide
Designated 2004-07-29. Orphan designation status: Positive.
First published: 2005-12-12. Last updated: 2016-08-17.

Intended use: Treatment of hepatic veno-occlusive disease

Source: https://www.ema.europa.eu/en/medicines/human/orphan-designations/eu-3-04-212